EMA orphan designation EU/3/20/2380
Active substance: allogeneic retinal pigment epithelial cells genetically modified with a non-viral vector to express beta-domain deleted human factor VIII
Designated 2020-12-09. Orphan designation status: Withdrawn.
First published: 2021-05-27. Last updated: 2022-12-05.

Intended use: Treatment of haemophilia A

Source: https://www.ema.europa.eu/en/medicines/human/orphan-designations/eu-3-20-2380